EMA orphan designation EU/3/15/1510: Radicava
Active substance: edaravone
Designated 2015-06-19. Orphan designation status: Positive. EMA product number: EMEA/H/C/004938.
First published: 2015-07-27. Last updated: 2023-07-21.

Intended use: Treatment of amyotrophic lateral sclerosis

Source: https://www.ema.europa.eu/en/medicines/human/orphan-designations/eu-3-15-1510